EMA orphan designation EU/3/22/2727: Deqtynet
Active substance: Copper (64Cu) oxodotreotide
Designated 2022-12-09. Orphan designation status: Positive. EMA product number: EMEA/H/C/006608.
First published: 2023-03-13. Last updated: 2026-05-22.

Intended use: Diagnosis of neuroendocrine neoplasms

Source: https://www.ema.europa.eu/en/medicines/human/orphan-designations/eu-3-22-2727